EMA orphan designation EU/3/21/2557
Active substance: insulin human
Designated 2022-01-14. Orphan designation status: Positive.
First published: 2022-06-09.

Intended use: Prevention of retinopathy of prematurity

Source: https://www.ema.europa.eu/en/medicines/human/orphan-designations/eu-3-21-2557